EMA orphan designation EU/3/24/2989
Active substance: avenciguat
Designated 2024-11-11. Orphan designation status: Withdrawn.
First published: 2024-11-21. Last updated: 2026-05-18.

Intended use: Treatment of systemic sclerosis

Source: https://www.ema.europa.eu/en/medicines/human/orphan-designations/eu-3-24-2989